EMA orphan designation EU/3/22/2733
Active substance: briquilimab
Designated 2022-12-09. Orphan designation status: Positive.
First published: 2023-03-13.

Intended use: Treatment in haematopoietic stem cell transplantation

Source: https://www.ema.europa.eu/en/medicines/human/orphan-designations/eu-3-22-2733